EMA orphan designation EU/3/25/3120
Active substance: rilzabrutinib
Designated 2025-08-22. Orphan designation status: Positive.
First published: 2026-04-10.

Intended use: Treatment of immunoglobulin G4-related disease

Source: https://www.ema.europa.eu/en/medicines/human/orphan-designations/eu-3-25-3120